EMA orphan designation EU/3/14/1347
Active substance: pyridoxal 5'-phosphate
Designated 2014-10-15. Orphan designation status: Positive.
First published: 2014-11-12. Last updated: 2014-11-12.

Intended use: Treatment of pyridoxamine 5'-phosphate oxidase deficiency

Source: https://www.ema.europa.eu/en/medicines/human/orphan-designations/eu-3-14-1347